EMA orphan designation EU/3/06/401
Active substance: N-methyl D-(2,3,4,5,6-pentahydroxy-hexyl)-ammonium; 2-(3,5-dichloro-phenyl)-benzoxazole-6-carboxylate (tafamidis)
Designated 2006-08-28. Orphan designation status: Expired.
First published: 2009-04-02. Last updated: 2021-11-25.

Intended use: Treatment of familial amyloid polyneuropathy

Source: https://www.ema.europa.eu/en/medicines/human/orphan-designations/eu-3-06-401